EMA orphan designation EU/3/19/2235
Active substance: synthetic double-stranded siRNA oligonucleotide directed against SERPINA1 mRNA and containing four modified nucleosides which form a ligand cluster of four N-acetylgalactosamine residues
Designated 2019-12-16. Orphan designation status: Withdrawn.
First published: 2020-02-25. Last updated: 2023-02-24.

Intended use: Treatment of congenital alpha-1 antitrypsin deficiency

Source: https://www.ema.europa.eu/en/medicines/human/orphan-designations/eu-3-19-2235